EMA orphan designation EU/3/11/938
Active substance: adeno-associated viral vector containing the human factor IX gene
Designated 2012-01-11. Orphan designation status: Positive.
First published: 2012-02-01. Last updated: 2021-08-06.

Intended use: Treatment of haemophilia B

Source: https://www.ema.europa.eu/en/medicines/human/orphan-designations/eu-3-11-938